EMA orphan designation EU/3/14/1320
Active substance: recombinant human diamine oxidase
Designated 2014-08-22. Orphan designation status: Positive.
First published: 2014-10-01. Last updated: 2014-10-01.

Intended use: Treatment of mastocytosis

Source: https://www.ema.europa.eu/en/medicines/human/orphan-designations/eu-3-14-1320